EMA orphan designation EU/3/15/1550
Active substance: 3-pentylbenzeneacetic acid sodium salt
Designated 2015-10-09. Orphan designation status: Withdrawn.
First published: 2015-11-16. Last updated: 2022-02-28.

Intended use: Treatment of idiopathic pulmonary fibrosis

Source: https://www.ema.europa.eu/en/medicines/human/orphan-designations/eu-3-15-1550